EMA orphan designation EU/3/25/3064
Active substance: recombinant human ectonucleotide pyrophosphatase/phosphodiesterase 1 fused to the Fc fragment of IgG1
Designated 2025-05-22. Orphan designation status: Positive.
First published: 2025-06-24.

Intended use: Treatment of calciphylaxis

Source: https://www.ema.europa.eu/en/medicines/human/orphan-designations/eu-3-25-3064